EMA orphan designation EU/3/04/255
Active substance: sabarubicin
Designated 2004-12-21. Orphan designation status: Withdrawn.
First published: 2009-10-15. Last updated: 2009-10-15.

Intended use: Treatment of small cell lung cancer

Source: https://www.ema.europa.eu/en/medicines/human/orphan-designations/eu-3-04-255